EMA orphan designation EU/3/10/771
Active substance: abarelix
Designated 2010-09-20. Orphan designation status: Withdrawn.
First published: 2010-10-18. Last updated: 2013-10-17.

Intended use: Treatment of low-flow priapism

Source: https://www.ema.europa.eu/en/medicines/human/orphan-designations/eu-3-10-771